EMA orphan designation EU/3/06/411
Active substance: recombinant fusion protein consisting of the extracellular portion of CD95 fused to the Fc part of a human IgG1 molecule
Designated 2006-10-31. Orphan designation status: Withdrawn.
First published: 2009-04-02. Last updated: 2023-09-15.

Intended use: Prevention of graft-versus-host disease

Source: https://www.ema.europa.eu/en/medicines/human/orphan-designations/eu-3-06-411